EMA orphan designation EU/3/11/885
Active substance: mixture of seven synthetic fragments consisting of p21 RAS peptides
Designated 2011-08-05. Orphan designation status: Positive.
First published: 2011-08-16. Last updated: 2023-09-13.

Intended use: Treatment of pancreatic cancer

Source: https://www.ema.europa.eu/en/medicines/human/orphan-designations/eu-3-11-885